EMA orphan designation EU/3/13/1102
Active substance: Cyclo-Cys-Gly-Gln-Arg-Glu-Thr-Pro-Glu-Gly-Ala-Glu-Ala-Lys-Pro-Trp-Tyr-Cys
Designated 2013-02-08. Orphan designation status: Positive.
First published: 2013-03-04. Last updated: 2013-03-04.

Intended use: Treatment of high-altitude pulmonary oedema

Source: https://www.ema.europa.eu/en/medicines/human/orphan-designations/eu-3-13-1102